EMA orphan designation EU/3/10/837
Active substance: plitidepsin
Designated 2011-02-23. Orphan designation status: Withdrawn.
First published: 2011-03-07. Last updated: 2011-09-06.

Intended use: Treatment of primary myelofibrosis

Source: https://www.ema.europa.eu/en/medicines/human/orphan-designations/eu-3-10-837